EMA orphan designation EU/3/06/407
Active substance: heparin-binding epidermal growth factor-like growth factor, amino acids 74-148
Designated 2006-10-31. Orphan designation status: Positive.
First published: 2009-04-02. Last updated: 2014-01-13.

Intended use: Prevention of necrotising enterocolitis

Source: https://www.ema.europa.eu/en/medicines/human/orphan-designations/eu-3-06-407